EMA orphan designation EU/3/21/2471
Active substance: humanised IgG2k Fc-modified bispecific monoclonal antibody against CD3 and BCMA
Designated 2021-07-19. Orphan designation status: Withdrawn.
First published: 2021-11-16. Last updated: 2026-05-26.

Intended use: Treatment of multiple myeloma

Source: https://www.ema.europa.eu/en/medicines/human/orphan-designations/eu-3-21-2471